EMA orphan designation EU/3/16/1668
Active substance: sodium nitrite; ethylenediaminetetraacetic acid
Designated 2016-05-30. Orphan designation status: Positive.
First published: 2016-06-22. Last updated: 2016-06-22.

Intended use: Treatment of cystic fibrosis

Source: https://www.ema.europa.eu/en/medicines/human/orphan-designations/eu-3-16-1668